EMA orphan designation EU/3/02/119
Active substance: Iodine (131I) anti-nucleohistone H1 chimeric biotinylated monoclonal antibody
Designated 2002-11-13. Orphan designation status: Withdrawn.
First published: 2003-05-26. Last updated: 2016-10-20.

Intended use: Treatment of glioma

Source: https://www.ema.europa.eu/en/medicines/human/orphan-designations/eu-3-02-119